EMA orphan designation EU/3/10/829
Active substance: tesetaxel
Designated 2010-12-17. Orphan designation status: Positive.
First published: 2011-01-20. Last updated: 2011-01-20.

Intended use: Treatment of gastric cancer

Source: https://www.ema.europa.eu/en/medicines/human/orphan-designations/eu-3-10-829